EMA orphan designation EU/3/20/2303
Active substance: adeno-associated viral vector expressing acid alpha-glucosidase gene
Designated 2020-07-27. Orphan designation status: Positive.
First published: 2020-11-19. Last updated: 2020-12-08.

Intended use: Treatment of glycogen storage disease type II (Pompe's disease)

Source: https://www.ema.europa.eu/en/medicines/human/orphan-designations/eu-3-20-2303